EMA orphan designation EU/3/24/2931
Active substance: nerandomilast
Designated 2024-06-28. Orphan designation status: Withdrawn.
First published: 2024-08-22. Last updated: 2025-06-10.

Intended use: Treatment of idiopathic pulmonary fibrosis

Source: https://www.ema.europa.eu/en/medicines/human/orphan-designations/eu-3-24-2931